EMA orphan designation EU/3/15/1466
Active substance: ex-vivo-expanded autologous human keratinocytes containing epidermal stem cells transduced with a COL7A1-encoding retroviral vector
Designated 2015-03-19. Orphan designation status: Positive.
First published: 2015-05-11. Last updated: 2015-05-11.

Intended use: Treatment of epidermolysis bullosa

Source: https://www.ema.europa.eu/en/medicines/human/orphan-designations/eu-3-15-1466